EMA orphan designation EU/3/19/2214: Ogsiveo
Active substance: nirogacestat
Designated 2019-10-17. Orphan designation status: Positive. EMA product number: EMEA/H/C/006071.
First published: 2020-01-23. Last updated: 2024-04-08.

Intended use: Treatment of soft tissue sarcoma

Source: https://www.ema.europa.eu/en/medicines/human/orphan-designations/eu-3-19-2214